EMA orphan designation EU/3/18/2027
Active substance: three human monoclonal antibodies against the EBOV glycoprotein
Designated 2018-05-25. Orphan designation status: Positive.
First published: 2018-07-23. Last updated: 2021-10-07.

Intended use: Treatment of Ebola virus disease

Source: https://www.ema.europa.eu/en/medicines/human/orphan-designations/eu-3-18-2027